EMA orphan designation EU/3/21/2410
Active substance: 2-[6-(6,7-Dimethoxyquinolin-3-yl)pyridin-3-yl]-N-[3-(1,1,1-trifluoro-2-methylpropan-2-yl)-1,2-oxazol-5-yl]acetamide (zeteletinib)
Designated 2021-02-19. Orphan designation status: Withdrawn.
First published: 2022-02-23. Last updated: 2025-09-04.

Intended use: Treatment of medullary thyroid carcinoma

Source: https://www.ema.europa.eu/en/medicines/human/orphan-designations/eu-3-21-2410